EMA orphan designation EU/3/08/569
Active substance: pegylated L-asparaginase
Designated 2008-09-22. Orphan designation status: Positive.
First published: 2009-06-29. Last updated: 2013-03-13.

Intended use: Treatment of acute lymphoblastic leukaemia

Source: https://www.ema.europa.eu/en/medicines/human/orphan-designations/eu-3-08-569